EMA orphan designation EU/3/16/1674
Active substance: allogeneic donor-derived ex-vivo expanded T lymphocytes transduced with a retroviral vector containing inducible caspase 9 and truncated CD19
Designated 2016-06-27. Orphan designation status: Withdrawn.
First published: 2016-07-26. Last updated: 2022-04-07.

Intended use: Treatment in haematopoietic stem cell transplantation

Source: https://www.ema.europa.eu/en/medicines/human/orphan-designations/eu-3-16-1674